EMA orphan designation EU/3/08/573
Active substance: adeno-associated viral vector containing the human alpha-sarcoglycan gene
Designated 2008-11-07. Orphan designation status: Withdrawn.
First published: 2009-04-02. Last updated: 2014-06-18.

Intended use: Treatment of alpha-sarcoglycanopathy

Source: https://www.ema.europa.eu/en/medicines/human/orphan-designations/eu-3-08-573